EMA orphan designation EU/3/12/1073
Active substance: Maytansinoid-conjugated human monoclonal antibody against mesothelin (anetumab ravtansine)
Designated 2012-12-06. Orphan designation status: Withdrawn.
First published: 2013-01-24. Last updated: 2018-10-24.

Intended use: Treatment of malignant mesothelioma

Source: https://www.ema.europa.eu/en/medicines/human/orphan-designations/eu-3-12-1073